EMA orphan designation EU/3/08/532
Active substance: filgrastim
Designated 2008-04-01. Orphan designation status: Positive.
First published: 2008-07-10. Last updated: 2015-03-26.

Intended use: Treatment of amyotrophic lateral sclerosis

Source: https://www.ema.europa.eu/en/medicines/human/orphan-designations/eu-3-08-532